EMA orphan designation EU/3/17/1931
Active substance: siplizumab
Designated 2017-10-16. Orphan designation status: Positive.
First published: 2018-01-09. Last updated: 2019-05-23.

Intended use: Treatment in solid organ transplantation

Source: https://www.ema.europa.eu/en/medicines/human/orphan-designations/eu-3-17-1931